EMA orphan designation EU/3/19/2176: Altuvoct
Active substance: recombinant human coagulation factor VIII Fc - von Willebrand factor - XTEN fusion protein
Designated 2019-06-28. Orphan designation status: Positive. EMA product number: EMEA/H/C/005968.
First published: 2019-10-14.

Intended use: Treatment of haemophilia A

Source: https://www.ema.europa.eu/en/medicines/human/orphan-designations/eu-3-19-2176